EMA orphan designation EU/3/04/241
Active substance: Pirfenidone
Designated 2004-11-16. Orphan designation status: Expired.
First published: 2009-09-23. Last updated: 2021-03-22.

Intended use: Treatment of idiopathic pulmonary fibrosis

Source: https://www.ema.europa.eu/en/medicines/human/orphan-designations/eu-3-04-241